EMA orphan designation EU/3/17/1933
Designated 2017-10-12. Orphan designation status: Positive.
First published: 2018-01-15. Last updated: 2018-12-07.

Intended use: Treatment of systemic sclerosis

Source: https://www.ema.europa.eu/en/medicines/human/orphan-designations/eu-3-17-1933